EMA orphan designation EU/3/05/284
Active substance: sodium butyrate
Designated 2005-05-27. Orphan designation status: Positive.
First published: 2005-06-29. Last updated: 2005-06-29.

Intended use: Prevention of radiation proctitis

Source: https://www.ema.europa.eu/en/medicines/human/orphan-designations/eu-3-05-284